EMA orphan designation EU/3/18/1999: Hemgenix
Active substance: recombinant adeno-associated viral vector containing a codon-optimized Padua derivative of human coagulation factor IX cDNA (etranacogene dezaparvovec)
Designated 2018-03-21. Orphan designation status: Positive. EMA product number: EMEA/H/C/004827.
First published: 2018-05-17. Last updated: 2021-07-01.

Intended use: Treatment of haemophilia B

Source: https://www.ema.europa.eu/en/medicines/human/orphan-designations/eu-3-18-1999